EMA orphan designation EU/3/05/295
Active substance: Hepatitis C immunoglobulin
Designated 2005-07-08. Orphan designation status: Positive.
First published: 2008-08-18. Last updated: 2008-08-18.

Intended use: Prevention of recurrent hepatitis C virus induced liver disease in liver transplant recipients

Source: https://www.ema.europa.eu/en/medicines/human/orphan-designations/eu-3-05-295